EMA orphan designation EU/3/05/337
Active substance: heparin sodium
Designated 2005-12-23. Orphan designation status: Withdrawn.
First published: 2006-08-08. Last updated: 2014-01-22.

Intended use: Treatment of cystic fibrosis

Source: https://www.ema.europa.eu/en/medicines/human/orphan-designations/eu-3-05-337